EMA orphan designation EU/3/06/429
Active substance: recombinant modified vaccinia Ankara expressing human 5T4
Designated 2007-01-26. Orphan designation status: Positive.
First published: 2007-12-04. Last updated: 2007-12-04.

Intended use: Treatment of renal-cell carcinoma

Source: https://www.ema.europa.eu/en/medicines/human/orphan-designations/eu-3-06-429